EMA orphan designation EU/3/25/3150
Active substance: surovatamig
Designated 2025-11-21. Orphan designation status: Positive.
First published: 2026-03-27.

Intended use: Treatment of B-lymphoblastic leukaemia/lymphoma

Source: https://www.ema.europa.eu/en/medicines/human/orphan-designations/eu-3-25-3150